EMA orphan designation EU/3/01/026
Active substance: L-Lysine-N-Acetyl-L-Cysteinate
Designated 2001-02-14. Orphan designation status: Positive.
First published: 2009-05-15. Last updated: 2009-05-15.

Intended use: Treatment of cystic fibrosis

Source: https://www.ema.europa.eu/en/medicines/human/orphan-designations/eu-3-01-026